EMA orphan designation EU/3/14/1247
Active substance: autologous dendritic cells pulsed with tumour antigen-derived synthetic peptides (MAGE-1, HER-2, AIM-2, TRP-2, gp-100, and interleukin-13 receptor alpha)
Designated 2014-02-19. Orphan designation status: Positive.
First published: 2014-04-02. Last updated: 2020-02-19.

Intended use: Treatment of glioma

Source: https://www.ema.europa.eu/en/medicines/human/orphan-designations/eu-3-14-1247